EMA orphan designation EU/3/22/2709
Active substance: ropeginterferon alfa-2b
Designated 2022-10-11. Orphan designation status: Positive.
First published: 2023-01-24.

Intended use: Treatment of essential thrombocythaemia

Source: https://www.ema.europa.eu/en/medicines/human/orphan-designations/eu-3-22-2709